EMA orphan designation EU/3/14/1294
Active substance: sodium acetate salt of the synthetic peptide H-D-Ala-Ser-Pro-Met-Leu-Val-Ala-Tyr-Asp-D-Ala-OH
Designated 2014-07-29. Orphan designation status: Positive.
First published: 2014-09-01. Last updated: 2015-05-13.

Intended use: Treatment of necrotising soft tissue infections

Source: https://www.ema.europa.eu/en/medicines/human/orphan-designations/eu-3-14-1294